EMA orphan designation EU/3/02/091
Active substance: TGF-ß2-specific phosphorothioate antisense oligodeoxynucleotide
Designated 2002-03-22. Orphan designation status: Positive.
First published: 2003-01-08. Last updated: 2022-03-18.

Intended use: Treatment of high-grade glioma

Source: https://www.ema.europa.eu/en/medicines/human/orphan-designations/eu-3-02-091